EMA orphan designation EU/3/09/634
Active substance: S-[2,3-bispalmitoyloxy-(2R)-propyl]-cysteinyl-GNNDESNISFKEK
Designated 2009-05-15. Orphan designation status: Positive.
First published: 2009-06-09. Last updated: 2009-06-09.

Intended use: Treatment of pancreatic cancer

Source: https://www.ema.europa.eu/en/medicines/human/orphan-designations/eu-3-09-634